EMA orphan designation EU/3/15/1572
Active substance: autologous T cells transduced with retroviral vector encoding an anti-CD19 CD28/CD3-zeta chimeric antigen receptor (axicabtagene ciloleucel)
Designated 2015-11-11. Orphan designation status: Withdrawn.
First published: 2016-01-06. Last updated: 2022-01-12.

Intended use: Treatment of chronic lymphocytic leukaemia / small lymphocytic lymphoma

Source: https://www.ema.europa.eu/en/medicines/human/orphan-designations/eu-3-15-1572